EMA orphan designation EU/3/25/3168
Active substance: domvanalimab
Designated 2025-09-12. Orphan designation status: Positive.
First published: 2026-03-26.

Intended use: Treatment of oesophageal cancer

Source: https://www.ema.europa.eu/en/medicines/human/orphan-designations/eu-3-25-3168